EMA orphan designation EU/3/20/2396
Active substance: alpha galactosidase A
Designated 2021-01-06. Orphan designation status: Positive.
First published: 2021-05-18.

Intended use: Treatment of Fabry disease

Source: https://www.ema.europa.eu/en/medicines/human/orphan-designations/eu-3-20-2396